EMA orphan designation EU/3/18/2012: Vyjuvek
Active substance: genetically modified replication-incompetent herpes simplex virus-1 expressing collagen VII (beremagene geperpavec)
Designated 2018-04-16. Orphan designation status: Positive. EMA product number: EMEA/H/C/006330.
First published: 2018-05-17. Last updated: 2025-06-10.

Intended use: Treatment of epidermolysis bullosa

Source: https://www.ema.europa.eu/en/medicines/human/orphan-designations/eu-3-18-2012